EMA orphan designation EU/3/16/1660
Active substance: autologous CD34+ cells transduced with lentiviral vector encoding the human beta globin gene
Designated 2016-05-30. Orphan designation status: Positive.
First published: 2016-06-23. Last updated: 2022-09-26.

Intended use: Treatment of beta thalassaemia intermedia and major

Source: https://www.ema.europa.eu/en/medicines/human/orphan-designations/eu-3-16-1660